EMA orphan designation EU/3/16/1610
Active substance: arsenic trioxide
Designated 2016-02-17. Orphan designation status: Positive.
First published: 2016-04-06. Last updated: 2016-04-06.

Intended use: Treatment of acute myeloid leukaemia

Source: https://www.ema.europa.eu/en/medicines/human/orphan-designations/eu-3-16-1610